EMA orphan designation EU/3/12/962
Active substance: chimeric monoclonal antibody against kappa myeloma antigen
Designated 2012-05-22. Orphan designation status: Withdrawn.
First published: 2012-07-06. Last updated: 2015-06-08.

Intended use: Treatment of multiple myeloma

Source: https://www.ema.europa.eu/en/medicines/human/orphan-designations/eu-3-12-962